EMA orphan designation EU/3/15/1582
Active substance: Magrolimab
Designated 2015-11-11. Orphan designation status: Withdrawn.
First published: 2016-01-06. Last updated: 2025-03-12.

Intended use: Treatment of acute myeloid leukaemia

Source: https://www.ema.europa.eu/en/medicines/human/orphan-designations/eu-3-15-1582